EMA orphan designation EU/3/18/2001
Active substance: recombinant modified ricin toxin A-chain subunit
Designated 2018-03-21. Orphan designation status: Positive.
First published: 2018-05-17. Last updated: 2020-06-08.

Intended use: Prevention of ricin poisoning

Source: https://www.ema.europa.eu/en/medicines/human/orphan-designations/eu-3-18-2001